EMA orphan designation EU/3/11/861
Active substance: 9-cis-Retinyl acetate
Designated 2011-05-13. Orphan designation status: Positive.
First published: 2011-05-27. Last updated: 2019-05-13.

Intended use: Treatment of Leber's congenital amaurosis

Source: https://www.ema.europa.eu/en/medicines/human/orphan-designations/eu-3-11-861